EMA orphan designation EU/3/16/1647
Active substance: Fluocinolone acetonide
Designated 2016-04-28. Orphan designation status: Withdrawn.
First published: 2016-05-30. Last updated: 2017-08-16.

Intended use: Treatment of non-infectious uveitis

Source: https://www.ema.europa.eu/en/medicines/human/orphan-designations/eu-3-16-1647